EMA orphan designation EU/3/08/605
Active substance: recombinant human monoclonal antibody to human Nogo-A protein of the IgG4/kappa class (atinumab)
Designated 2009-01-19. Orphan designation status: Withdrawn.
First published: 2009-05-15. Last updated: 2019-10-16.

Intended use: Treatment of spinal cord injury

Source: https://www.ema.europa.eu/en/medicines/human/orphan-designations/eu-3-08-605